EMA orphan designation EU/3/12/1070
Active substance: recombinant human dyskerin
Designated 2012-11-08. Orphan designation status: Positive.
First published: 2012-12-12. Last updated: 2017-01-05.

Intended use: Treatment of dyskeratosis congenita

Source: https://www.ema.europa.eu/en/medicines/human/orphan-designations/eu-3-12-1070